EMA orphan designation EU/3/15/1464
Active substance: autologous adipose tissue-derived stromal vascular fraction cells
Designated 2015-03-19. Orphan designation status: Positive.
First published: 2015-05-11. Last updated: 2015-05-11.

Intended use: Treatment of systemic sclerosis

Source: https://www.ema.europa.eu/en/medicines/human/orphan-designations/eu-3-15-1464